EMA orphan designation EU/3/02/094
Active substance: chimeric IgG monoclonal antibody cG250 (girentuximab)
Designated 2002-03-19. Orphan designation status: Withdrawn.
First published: 2003-01-08. Last updated: 2021-09-02.

Intended use: Treatment of renal-cell carcinoma

Source: https://www.ema.europa.eu/en/medicines/human/orphan-designations/eu-3-02-094